EMA orphan designation EU/3/18/2119
Active substance: Marizomib
Designated 2018-12-14. Orphan designation status: Positive.
First published: 2019-03-12.

Intended use: Treatment of glioma

Source: https://www.ema.europa.eu/en/medicines/human/orphan-designations/eu-3-18-2119